EMA orphan designation EU/3/16/1826: Idefirix
Active substance: recombinant IgG degrading enzyme of Streptococcus pyogenes (imlifidase)
Designated 2017-01-12. Orphan designation status: Positive. EMA product number: EMEA/H/C/004849.
First published: 2017-07-14. Last updated: 2020-08-28.

Intended use: Prevention of graft rejection following solid organ transplantation

Source: https://www.ema.europa.eu/en/medicines/human/orphan-designations/eu-3-16-1826